EMA orphan designation EU/3/07/430
Active substance: artesunate
Designated 2007-02-20. Orphan designation status: Positive.
First published: 2008-07-10. Last updated: 2008-07-10.

Intended use: Treatment of malaria

Source: https://www.ema.europa.eu/en/medicines/human/orphan-designations/eu-3-07-430